EMA orphan designation EU/3/05/331
Active substance: recombinant microbial lipase
Designated 2005-10-28. Orphan designation status: Withdrawn.
First published: 2006-03-13. Last updated: 2013-03-01.

Intended use: Treatment of malabsorption due to exocrine pancreatic enzyme insufficiency

Source: https://www.ema.europa.eu/en/medicines/human/orphan-designations/eu-3-05-331